EMA orphan designation EU/3/16/1719
Active substance: cisplatin
Designated 2016-08-29. Orphan designation status: Positive.
First published: 2016-10-03. Last updated: 2016-10-03.

Intended use: Treatment of malignant mesothelioma

Source: https://www.ema.europa.eu/en/medicines/human/orphan-designations/eu-3-16-1719